EMA orphan designation EU/3/14/1312
Active substance: recombinant factor VIIa modified with three terminal repeats derived from the β chain of human chorionic gonadotropin
Designated 2014-08-22. Orphan designation status: Positive.
First published: 2014-09-30. Last updated: 2021-09-16.

Intended use: Treatment of congenital factor VII deficiency

Source: https://www.ema.europa.eu/en/medicines/human/orphan-designations/eu-3-14-1312